EMA orphan designation EU/3/16/1652
Active substance: recombinant human cerebral dopamine neurotrophic factor
Designated 2016-04-28. Orphan designation status: Positive.
First published: 2016-05-30. Last updated: 2016-05-30.

Intended use: Treatment of amyotrophic lateral sclerosis

Source: https://www.ema.europa.eu/en/medicines/human/orphan-designations/eu-3-16-1652